EMA orphan designation EU/3/03/151
Active substance: aplidine
Designated 2003-07-09. Orphan designation status: Withdrawn.
First published: 2005-12-12. Last updated: 2011-09-06.

Intended use: Treatment of acute lymphoblastic leukaemia

Source: https://www.ema.europa.eu/en/medicines/human/orphan-designations/eu-3-03-151